EMA orphan designation EU/3/15/1436
Active substance: Lactobacillus reuteri
Designated 2015-02-12. Orphan designation status: Positive.
First published: 2015-04-09. Last updated: 2015-04-09.

Intended use: Prevention of necrotising enterocolitis

Source: https://www.ema.europa.eu/en/medicines/human/orphan-designations/eu-3-15-1436